EMA orphan designation EU/3/16/1806
Active substance: recombinant self-complementary adeno-associated viral vector serotype 9 containing the human CLN3 gene
Orphan designation status: Withdrawn.
First published: 2017-01-09. Last updated: 2023-06-20.

Intended use: Treatment of neuronal ceroid lipofuscinosis

Source: https://www.ema.europa.eu/en/medicines/human/orphan-designations/eu-3-16-1806